EMA orphan designation EU/3/08/559
Active substance: Bosentan
Designated 2008-09-05. Orphan designation status: Withdrawn.
First published: 2009-04-02. Last updated: 2014-05-08.

Intended use: Treatment of idiopathic pulmonary fibrosis

Source: https://www.ema.europa.eu/en/medicines/human/orphan-designations/eu-3-08-559